EMA orphan designation EU/3/17/1904
Active substance: Picropodophyllin
Designated 2017-08-23. Orphan designation status: Positive.
First published: 2017-10-17. Last updated: 2020-11-27.

Intended use: Treatment of glioma

Source: https://www.ema.europa.eu/en/medicines/human/orphan-designations/eu-3-17-1904